EMA orphan designation EU/3/03/174
Active substance: Gimatecan
Designated 2003-12-01. Orphan designation status: Positive.
First published: 2009-09-21. Last updated: 2009-09-21.

Intended use: Treatment of glioma

Source: https://www.ema.europa.eu/en/medicines/human/orphan-designations/eu-3-03-174